EMA orphan designation EU/3/18/2108
Active substance: adeno-associated viral vector expressing human 21- hydroxylase
Designated 2018-12-14. Orphan designation status: Positive.
First published: 2019-03-07.

Intended use: Treatment of congenital adrenal hyperplasia

Source: https://www.ema.europa.eu/en/medicines/human/orphan-designations/eu-3-18-2108